EMA orphan designation EU/3/11/935
Active substance: Interferon gamma
Designated 2011-12-09. Orphan designation status: Withdrawn.
First published: 2011-12-20. Last updated: 2025-09-04.

Intended use: Treatment of Friedreich's ataxia

Source: https://www.ema.europa.eu/en/medicines/human/orphan-designations/eu-3-11-935